EMA orphan designation EU/3/23/2814
Active substance: tarperprumig
Designated 2023-07-25. Orphan designation status: Positive.
First published: 2024-02-01.

Intended use: Treatment of sickle cell disease

Source: https://www.ema.europa.eu/en/medicines/human/orphan-designations/eu-3-23-2814